EMA orphan designation EU/3/14/1268
Active substance: Humanised monoclonal antibody against CD38 for the treatment of plasma cell myeloma (isatuximab)
Designated 2014-04-29. Orphan designation status: Withdrawn.
First published: 2014-06-04. Last updated: 2020-06-12.

Intended use: Treatment of plasma cell myeloma

Source: https://www.ema.europa.eu/en/medicines/human/orphan-designations/eu-3-14-1268